EMA orphan designation EU/3/24/2898
Active substance: donidalorsen
Designated 2024-02-19. Orphan designation status: Withdrawn.
First published: 2024-04-11. Last updated: 2024-10-22.

Intended use: Treatment of hereditary angioedema

Source: https://www.ema.europa.eu/en/medicines/human/orphan-designations/eu-3-24-2898